EMA orphan designation EU/3/15/1497
Active substance: 5,7-dichloro-2-dimethylaminomethyl-8-hydroxyquinoline hydrochloride
Designated 2015-05-21. Orphan designation status: Withdrawn.
First published: 2015-06-30. Last updated: 2019-11-20.

Intended use: Treatment of Huntington’s disease

Source: https://www.ema.europa.eu/en/medicines/human/orphan-designations/eu-3-15-1497